EMA orphan designation EU/3/14/1394
Active substance: pegylated recombinant human hyaluronidase PH20(pegvorhyaluronidase alfa)
Designated 2014-12-16. Orphan designation status: Withdrawn.
First published: 2015-02-26. Last updated: 2019-02-22.

Intended use: Treatment of pancreatic cancer

Source: https://www.ema.europa.eu/en/medicines/human/orphan-designations/eu-3-14-1394